EMA orphan designation EU/3/07/452
Active substance: R-1-[2,3-dihydro-2-oxo-1-pivaloylmethyl-5-(2-pyridyl)-1 H-1,4-benzodiazepin-3-yl]-3-(3-methylaminophenyl)urea (netazepide)
Designated 2007-06-14. Orphan designation status: Positive.
First published: 2007-07-19. Last updated: 2021-03-02.

Intended use: Treatment of gastric carcinoid

Source: https://www.ema.europa.eu/en/medicines/human/orphan-designations/eu-3-07-452